EMA orphan designation EU/3/21/2493
Active substance: autologous CD34+ haematopoietic stem and progenitor cells genetically modified with the lentiviral vector encoding for the human iduronate 2-sulfatase gene
Designated 2021-08-20. Orphan designation status: Positive.
First published: 2022-03-02.

Intended use: Treatment of mucopolysaccharidosis type II (Hunter's syndrome)

Source: https://www.ema.europa.eu/en/medicines/human/orphan-designations/eu-3-21-2493